EMA orphan designation EU/3/22/2729
Active substance: recombinant adeno-associated virus Olig001 containing human aspartoacylase cDNA
Designated 2022-12-09. Orphan designation status: Positive.
First published: 2023-03-13.

Intended use: Treatment of Canavan disease

Source: https://www.ema.europa.eu/en/medicines/human/orphan-designations/eu-3-22-2729